EMA orphan designation EU/3/09/702
Active substance: beta-artemether; lumefantrine
Designated 2010-01-28. Orphan designation status: Positive.
First published: 2010-02-25. Last updated: 2010-02-25.

Intended use: Treatment of malaria

Source: https://www.ema.europa.eu/en/medicines/human/orphan-designations/eu-3-09-702